EMA orphan designation EU/3/16/1752
Active substance: human monoclonal IgG1 antibody against tissue factor pathway inhibitor
Designated 2016-10-14. Orphan designation status: Withdrawn.
First published: 2016-11-14. Last updated: 2024-12-03.

Intended use: Treatment of haemophilia A

Source: https://www.ema.europa.eu/en/medicines/human/orphan-designations/eu-3-16-1752